EMA orphan designation EU/3/11/928
Active substance: cysteamine
Designated 2011-12-09. Orphan designation status: Positive.
First published: 2011-12-20. Last updated: 2019-05-21.

Intended use: Treatment of cystic fibrosis

Source: https://www.ema.europa.eu/en/medicines/human/orphan-designations/eu-3-11-928